EMA orphan designation EU/3/18/2047
Active substance: liposomal mannose-1-phosphate
Designated 2018-07-31. Orphan designation status: Positive.
First published: 2018-10-11. Last updated: 2020-05-07.

Intended use: treatment of phosphomannomutase 2-congenital disorder of glycosylation

Source: https://www.ema.europa.eu/en/medicines/human/orphan-designations/eu-3-18-2047